EMA orphan designation EU/3/21/2439
Active substance: melatonin
Designated 2021-05-20. Orphan designation status: Positive.
First published: 2022-03-18.

Intended use: Treatment of retinitis pigmentosa

Source: https://www.ema.europa.eu/en/medicines/human/orphan-designations/eu-3-21-2439